EMA orphan designation EU/3/11/886: Omjjara
Active substance: N-(cyanomethyl)-4-(2-{[4-(morpholin-4-yl)phenyl]amino}pyrimidin-4-yl)benzamide, dihydrochloride salt (momelotinib)
Designated 2011-08-05. Orphan designation status: Positive. EMA product number: EMEA/H/C/005768.
First published: 2011-08-16. Last updated: 2022-10-04.

Intended use: Treatment of post-polycythaemia vera myelofibrosis

Source: https://www.ema.europa.eu/en/medicines/human/orphan-designations/eu-3-11-886